EMA orphan designation EU/3/22/2681
Active substance: autologous human bone marrow-derived haematopoietic and mesenchymal stem cells depleted of erythrocytes, monocytes and lymphocytes
Designated 2022-08-10. Orphan designation status: Positive.
First published: 2022-12-15.

Intended use: Treatment of frontotemporal dementia

Source: https://www.ema.europa.eu/en/medicines/human/orphan-designations/eu-3-22-2681